EMA orphan designation EU/3/06/372
Active substance: hydrocortisone
Designated 2006-05-22. Orphan designation status: Expired.
First published: 2009-09-21. Last updated: 2021-11-25.

Intended use: Treatment of adrenal insufficiency

Source: https://www.ema.europa.eu/en/medicines/human/orphan-designations/eu-3-06-372